EMA orphan designation EU/3/12/1047
Active substance: Asp-Arg-Val-Tyr-Ile-His-Pro
Designated 2012-10-10. Orphan designation status: Withdrawn.
First published: 2012-11-16. Last updated: 2016-01-19.

Intended use: Treatment of acute lung Injury

Source: https://www.ema.europa.eu/en/medicines/human/orphan-designations/eu-3-12-1047